EMA orphan designation EU/3/23/2752
Active substance: humanised IgG1 monoclonal antibody against annexin-A1
Designated 2023-02-15. Orphan designation status: Positive.
First published: 2024-01-24. Last updated: 2024-10-22.

Intended use: Treatment of pancreatic cancer

Source: https://www.ema.europa.eu/en/medicines/human/orphan-designations/eu-3-23-2752